EMA orphan designation EU/3/16/1685
Active substance: molgramostim
Designated 2016-06-27. Orphan designation status: Positive.
First published: 2016-07-26. Last updated: 2022-04-06.

Intended use: Treatment of acute respiratory distress syndrome (ARDS)

Source: https://www.ema.europa.eu/en/medicines/human/orphan-designations/eu-3-16-1685